EMA orphan designation EU/3/07/512
Active substance: 17-(Allylamino)-17-demethoxygeldanamycin, hydroquinone hydrochloride
Designated 2007-11-29. Orphan designation status: Withdrawn.
First published: 2009-09-17. Last updated: 2011-03-25.

Intended use: Treatment of malignant gastrointestinal stromal tumours

Source: https://www.ema.europa.eu/en/medicines/human/orphan-designations/eu-3-07-512